EMA orphan designation EU/3/21/2527: Alyftrek
Active substance: (14S)-8-[3-(2-{dispiro[2.0.24.13]heptan-7-yl}ethoxy)-1H-pyrazol-1-yl]-12,12-dimethyl-2lamba6-thia-3,9,11,18,23-penta-azatetracyclo[17.3.1.111,14.05,10]tetracosa-1(22),5,7,9,19(23),20-hexaene-2,2,4-trione calcium salt hydrate, deutivacaftor, tezacaftor
Designated 2021-11-12. Orphan designation status: Positive. EMA product number: EMEA/H/C/006382.
First published: 2022-06-07. Last updated: 2025-04-25.

Intended use: Treatment of cystic fibrosis

Source: https://www.ema.europa.eu/en/medicines/human/orphan-designations/eu-3-21-2527